EMA orphan designation EU/3/15/1526
Active substance: triheptanoin
Designated 2015-07-28. Orphan designation status: Positive.
First published: 2015-08-18. Last updated: 2020-11-11.

Intended use: Treatment of carnitine palmitoyltransferase II deficiency

Source: https://www.ema.europa.eu/en/medicines/human/orphan-designations/eu-3-15-1526